EMA orphan designation EU/3/21/2453
Active substance: melatonin
Designated 2021-06-21. Orphan designation status: Positive.
First published: 2022-05-11.

Intended use: Prevention of retinopathy of prematurity

Source: https://www.ema.europa.eu/en/medicines/human/orphan-designations/eu-3-21-2453